EMA orphan designation EU/3/04/187
Active substance: human monoclonal hepatitis B immunoglobulins
Designated 2004-02-23. Orphan designation status: Withdrawn.
First published: 2004-07-10. Last updated: 2010-11-18.

Intended use: Prevention of hepatitis-B re-infection following liver transplantation

Source: https://www.ema.europa.eu/en/medicines/human/orphan-designations/eu-3-04-187